EMA orphan designation EU/3/25/3116
Active substance: alpibectir; ethionamide
Orphan designation status: Positive.
First published: 2026-03-25.

Intended use: Treatment of tuberculosis

Source: https://www.ema.europa.eu/en/medicines/human/orphan-designations/eu-3-25-3116